EMA orphan designation EU/3/07/440
Active substance: recombinant adeno-associated viral vector containing human alpha-1 antitrypsin gene
Designated 2007-03-20. Orphan designation status: Positive.
First published: 2007-07-19. Last updated: 2022-10-06.

Intended use: Treatment of congenital alpha-1 antitrypsin deficiency

Source: https://www.ema.europa.eu/en/medicines/human/orphan-designations/eu-3-07-440